EMA orphan designation EU/3/07/486
Active substance: adenovirus associated viral vector serotype 4 containing the human RPE65 gene
Designated 2007-11-14. Orphan designation status: Positive.
First published: 2008-07-02. Last updated: 2022-08-09.

Intended use: Treatment of retinitis pigmentosa

Source: https://www.ema.europa.eu/en/medicines/human/orphan-designations/eu-3-07-486